EMA orphan designation EU/3/17/1828: Symkevi
Active substance: ivacaftor; tezacaftor
Designated 2017-02-27. Orphan designation status: Positive. EMA product number: EMEA/H/C/004682.
First published: 2017-03-31. Last updated: 2022-01-25.

Intended use: Treatment of cystic fibrosis

Source: https://www.ema.europa.eu/en/medicines/human/orphan-designations/eu-3-17-1828